EMA orphan designation EU/3/12/1045
Active substance: alpha-1 proteinase inhibitor
Designated 2012-10-10. Orphan designation status: Positive.
First published: 2012-11-16. Last updated: 2012-11-16.

Intended use: Treatment of cystic fibrosis

Source: https://www.ema.europa.eu/en/medicines/human/orphan-designations/eu-3-12-1045